EMA orphan designation EU/3/18/2036
Active substance: L-cystine bis(N-methylpiperazide)
Designated 2018-06-27. Orphan designation status: Positive.
First published: 2018-08-21. Last updated: 2021-04-30.

Intended use: Treatment of cystinuria

Source: https://www.ema.europa.eu/en/medicines/human/orphan-designations/eu-3-18-2036